EMA orphan designation EU/3/25/3151
Active substance: leuconostoc citreum, strain G511, live
Designated 2025-11-21. Orphan designation status: Positive.
First published: 2026-04-10.

Intended use: Treatment of primary sclerosing cholangitis

Source: https://www.ema.europa.eu/en/medicines/human/orphan-designations/eu-3-25-3151